EMA orphan designation EU/3/05/305
Active substance: imatinib mesilate
Designated 2005-08-26. Orphan designation status: Expired.
First published: 2007-02-28. Last updated: 2015-02-09.

Intended use: Treatment of dermatofibrosarcoma protuberans

Source: https://www.ema.europa.eu/en/medicines/human/orphan-designations/eu-3-05-305